EMA orphan designation EU/3/18/2035
Active substance: Efpegsomatropin
Designated 2018-06-27. Orphan designation status: Positive.
First published: 2018-08-21. Last updated: 2019-05-23.

Intended use: Treatment of growth hormone deficiency

Source: https://www.ema.europa.eu/en/medicines/human/orphan-designations/eu-3-18-2035